EMA orphan designation EU/3/20/2288
Active substance: Magrolimab
Designated 2020-06-26. Orphan designation status: Withdrawn.
First published: 2020-09-22. Last updated: 2025-03-12.

Intended use: Treatment of myelodysplastic syndromes

Source: https://www.ema.europa.eu/en/medicines/human/orphan-designations/eu-3-20-2288